EMA orphan designation EU/3/25/3177
Active substance: rezatapopt
Designated 2025-12-09. Orphan designation status: Positive.
First published: 2026-03-27.

Intended use: Treatment of ovarian cancer

Source: https://www.ema.europa.eu/en/medicines/human/orphan-designations/eu-3-25-3177-0